EMA orphan designation EU/3/12/1032
Active substance: vatreptacog alfa (activated)
Designated 2012-08-09. Orphan designation status: Withdrawn.
First published: 2012-09-27. Last updated: 2015-03-06.

Intended use: Treatment of haemophilia B

Source: https://www.ema.europa.eu/en/medicines/human/orphan-designations/eu-3-12-1032